EMA orphan designation EU/3/25/3031
Active substance: adeno-associated virus sector serotype rh74 containing the human SGCG gene
Designated 2025-02-27. Orphan designation status: Positive.
First published: 2025-03-11.

Intended use: Treatment of limb-girdle muscular dystrophy

Source: https://www.ema.europa.eu/en/medicines/human/orphan-designations/eu-3-25-3031